EMA orphan designation EU/3/07/509
Active substance: azacitidine
Designated 2007-11-29. Orphan designation status: Expired.
First published: 2009-09-17. Last updated: 2019-02-20.

Intended use: Treatment of acute myeloid leukaemia

Source: https://www.ema.europa.eu/en/medicines/human/orphan-designations/eu-3-07-509